EMA orphan designation EU/3/12/978
Active substance: melatonin
Designated 2012-04-02. Orphan designation status: Positive.
First published: 2012-04-30. Last updated: 2024-04-08.

Intended use: Treatment of perinatal asphyxia

Source: https://www.ema.europa.eu/en/medicines/human/orphan-designations/eu-3-12-978